EMA orphan designation EU/3/06/379
Active substance: Diphenylcyclopropenone
Designated 2006-06-29. Orphan designation status: Withdrawn.
First published: 2009-04-24. Last updated: 2013-09-19.

Intended use: Treatment of alopecia totalis

Source: https://www.ema.europa.eu/en/medicines/human/orphan-designations/eu-3-06-379